EMA orphan designation EU/3/15/1560
Active substance: N-(2-((4Z,7Z,10Z,13Z,16Z,19Z)-docosa-4,7,10,13,16,19-hexaenamido)ethyl)-2-hydroxybenzamide (edasalonexent)
Designated 2015-10-09. Orphan designation status: Withdrawn.
First published: 2015-11-18. Last updated: 2021-09-02.

Intended use: Treatment of Duchenne muscular dystrophy

Source: https://www.ema.europa.eu/en/medicines/human/orphan-designations/eu-3-15-1560